EMA orphan designation EU/3/22/2705
Active substance: selinexor
Designated 2022-10-11. Orphan designation status: Positive.
First published: 2023-01-24. Last updated: 2026-05-11.

Intended use: Treatment of myelofibrosis

Source: https://www.ema.europa.eu/en/medicines/human/orphan-designations/eu-3-22-2705